EMA orphan designation EU/3/21/2454
Active substance: Humanised monoclonal antibody derivative against fibroblast growth factor receptor 3
Designated 2021-06-21. Orphan designation status: Positive.
First published: 2022-05-11. Last updated: 2023-07-18.

Intended use: Treatment of achondroplasia

Source: https://www.ema.europa.eu/en/medicines/human/orphan-designations/eu-3-21-2454